EMA orphan designation EU/3/20/2316
Active substance: protein-based delivery vector carrying a DNA payload encoding an RNA-guided nuclease that targets stx genes of Shiga toxin-producing Escherichia coli
Designated 2020-08-21. Orphan designation status: Positive.
First published: 2020-11-25. Last updated: 2022-06-22.

Intended use: Prevention of haemolytic uraemic syndrome

Source: https://www.ema.europa.eu/en/medicines/human/orphan-designations/eu-3-20-2316